EMA orphan designation EU/3/16/1624
Active substance: acalabrutinib
Designated 2016-03-21. Orphan designation status: Withdrawn.
First published: 2016-05-02. Last updated: 2020-11-11.

Intended use: Treatment of chronic lymphocytic leukaemia / small lymphocytic lymphoma

Source: https://www.ema.europa.eu/en/medicines/human/orphan-designations/eu-3-16-1624